EMA orphan designation EU/3/17/1835
Active substance: ex-vivo-expanded autologous keratinocytes transduced with retroviral vector containing the COL7A1 gene (prademagene zamikeracel)
Designated 2017-02-27. Orphan designation status: Positive.
First published: 2017-03-31. Last updated: 2023-01-30.

Intended use: Treatment of epidermolysis bullosa

Source: https://www.ema.europa.eu/en/medicines/human/orphan-designations/eu-3-17-1835